EMA orphan designation EU/3/04/230
Active substance: Dexamethasone sodium phosphate encapsulated in human erythrocytes
Designated 2004-10-20. Orphan designation status: Withdrawn.
First published: 2009-11-05. Last updated: 2026-05-18.

Intended use: Treatment of cystic fibrosis

Source: https://www.ema.europa.eu/en/medicines/human/orphan-designations/eu-3-04-230